EMA orphan designation EU/3/14/1389
Active substance: Adeno-associated viral vector serotype rh.rh.10 carrying the human N-sulfoglucosamine sulfohydrolase cDNA
Designated 2014-12-16. Orphan designation status: Positive.
First published: 2015-02-26. Last updated: 2015-05-13.

Intended use: Treatment of mucopolysaccharidosis, type IIIA (Sanfilippo A syndrome)

Source: https://www.ema.europa.eu/en/medicines/human/orphan-designations/eu-3-14-1389